EMA orphan designation EU/3/20/2390: Rilonacept FGK Representative Service GmbH
Active substance: rilonacept
Designated 2021-01-06. Orphan designation status: Positive. EMA product number: EMEA/H/C/006537.
First published: 2021-05-18. Last updated: 2024-10-23.

Intended use: Treatment of idiopathic pericarditis

Source: https://www.ema.europa.eu/en/medicines/human/orphan-designations/eu-3-20-2390